EMA orphan designation EU/3/04/237
Active substance: recombinant human insulin-like growth factor-I; recombinant human insulin-like growth factor binding protein-3
Designated 2004-10-21. Orphan designation status: Withdrawn.
First published: 2009-02-20. Last updated: 2011-06-21.

Intended use: Treatment of Rabson-Mendenhall syndrome

Source: https://www.ema.europa.eu/en/medicines/human/orphan-designations/eu-3-04-237